EMA orphan designation EU/3/15/1461
Active substance: recombinant human monoclonal antibody binding to vascular adhesion protein-1
Designated 2015-03-19. Orphan designation status: Positive.
First published: 2015-05-11. Last updated: 2022-07-08.

Intended use: Treatment of primary sclerosing cholangitis

Source: https://www.ema.europa.eu/en/medicines/human/orphan-designations/eu-3-15-1461